EMA orphan designation EU/3/21/2549
Active substance: Norrin (25-133), Lys86Pro
Designated 2021-12-10. Orphan designation status: Positive.
First published: 2022-05-03.

Intended use: Treatment of familial exudative vitreoretinopathy

Source: https://www.ema.europa.eu/en/medicines/human/orphan-designations/eu-3-21-2549